EMA orphan designation EU/3/19/2173
Active substance: Imidazolyl ethanamide pentandioic acid
Designated 2019-06-28. Orphan designation status: Positive.
First published: 2019-10-07. Last updated: 2020-05-04.

Intended use: Treatment of acute radiation syndrome

Source: https://www.ema.europa.eu/en/medicines/human/orphan-designations/eu-3-19-2173